EMA orphan designation EU/3/18/2033
Active substance: codon-optimised human ornithine transcarbamylase mRNA complexed with lipid-based nanoparticles
Designated 2018-06-27. Orphan designation status: Withdrawn.
First published: 2018-08-21. Last updated: 2021-04-28.

Intended use: Treatment of ornithine transcarbamylase deficiency

Source: https://www.ema.europa.eu/en/medicines/human/orphan-designations/eu-3-18-2033